EMA orphan designation EU/3/03/168
Active substance: Herpes simplex 1 virus-thymidine kinase and truncated low affinity nerve growth factor receptor transfected donor lymphocytes
Designated 2003-10-20. Orphan designation status: Withdrawn.
First published: 2006-01-04. Last updated: 2020-01-16.

Intended use: Adjunctive treatment in haematopoietic cell transplantation

Source: https://www.ema.europa.eu/en/medicines/human/orphan-designations/eu-3-03-168